EMA orphan designation EU/3/24/2990
Active substance: Mesenchymal stem cells-derived small extracellular vesicles loaded with siRNA against phosphatase and tensin homolog
Designated 2024-11-11. Orphan designation status: Positive.
First published: 2024-11-19.

Intended use: Treatment of spinal cord injury

Source: https://www.ema.europa.eu/en/medicines/human/orphan-designations/eu-3-24-2990